EMA orphan designation EU/3/17/1964
Active substance: Itacitinib
Designated 2018-01-17. Orphan designation status: Positive.
First published: 2018-03-13. Last updated: 2018-03-13.

Intended use: Treatment of graft-versus-host disease

Source: https://www.ema.europa.eu/en/medicines/human/orphan-designations/eu-3-17-1964